EMA orphan designation EU/3/24/2979
Active substance: navenibart
Designated 2024-11-10. Orphan designation status: Positive.
First published: 2024-11-20.

Intended use: Treatment of hereditary angioedema

Source: https://www.ema.europa.eu/en/medicines/human/orphan-designations/eu-3-24-2979